EMA orphan designation EU/3/01/070
Active substance: celecoxib
Designated 2001-11-21. Orphan designation status: Withdrawn.
First published: 2009-06-15. Last updated: 2019-02-21.

Intended use: Treatment of familial adenomatous polyposis (FAP)

Source: https://www.ema.europa.eu/en/medicines/human/orphan-designations/eu-3-01-070